EMA orphan designation EU/3/19/2224: Ztalmy
Active substance: ganaxolone
Designated 2019-11-13. Orphan designation status: Positive. EMA product number: EMEA/H/C/005825.
First published: 2020-02-13. Last updated: 2025-06-05.

Intended use: Treatment of CDKL5 deficiency disorder

Source: https://www.ema.europa.eu/en/medicines/human/orphan-designations/eu-3-19-2224